EMA orphan designation EU/3/15/1478
Active substance: rimeporide
Orphan designation status: Positive.
First published: 2015-05-21. Last updated: 2025-03-11.

Intended use: Treatment of Duchenne muscular dystrophy

Source: https://www.ema.europa.eu/en/medicines/human/orphan-designations/eu-3-15-1478